EMA orphan designation EU/3/22/2664
Active substance: human decorin fused to the truncated homing peptide CRK
Designated 2022-07-18. Orphan designation status: Positive.
First published: 2023-01-23. Last updated: 2025-06-05.

Intended use: Treatment of epidermolysis bullosa

Source: https://www.ema.europa.eu/en/medicines/human/orphan-designations/eu-3-22-2664